EMA orphan designation EU/3/12/1013
Active substance: recombinant human interleukin-7
Designated 2012-07-04. Orphan designation status: Positive.
First published: 2012-07-24. Last updated: 2014-07-14.

Intended use: Treatment of progressive multifocal leukoencephalopathy

Source: https://www.ema.europa.eu/en/medicines/human/orphan-designations/eu-3-12-1013